EMA orphan designation EU/3/21/2561
Active substance: chimeric peptide of human glucagon-like peptide-1, glucagon and gastric inhibitory polypeptide analogues linked to a human immunoglobulin Fc fragment
Designated 2022-01-14. Orphan designation status: Positive.
First published: 2022-06-15.

Intended use: Treatment of primary biliary cholangitis

Source: https://www.ema.europa.eu/en/medicines/human/orphan-designations/eu-3-21-2561